EMA orphan designation EU/3/14/1301
Active substance: Humanised anti-alpha ν beta 6 monoclonal antibody
Designated 2014-07-29. Orphan designation status: Positive.
First published: 2014-09-01. Last updated: 2023-04-03.

Intended use: Treatment of idiopathic pulmonary fibrosis

Source: https://www.ema.europa.eu/en/medicines/human/orphan-designations/eu-3-14-1301